EMA orphan designation EU/3/26/3207
Active substance: non-replicating adeno-associated virus serotype 5 containing the human retinal guanylate cyclase 1 gene
Designated 2026-02-23. Orphan designation status: Positive.
First published: 2026-04-14.

Intended use: Treatment of inherited retinal dystrophy due to biallelic autosomal recessive mutations in the human guanylate cyclase 1 (GUCY2D) gene

Source: https://www.ema.europa.eu/en/medicines/human/orphan-designations/eu-3-26-3207